EMA orphan designation EU/3/13/1188
Active substance: recombinant fusion protein linking coagulation factor VIIa with albumin
Designated 2013-10-07. Orphan designation status: Positive.
First published: 2013-10-31. Last updated: 2018-03-28.

Intended use: Treatment of congenital factor VII deficiency

Source: https://www.ema.europa.eu/en/medicines/human/orphan-designations/eu-3-13-1188